EMA orphan designation EU/3/05/338
Active substance: dasatinib
Designated 2005-12-23. Orphan designation status: Expired.
First published: 2007-02-28. Last updated: 2017-02-08.

Intended use: Treatment of acute lymphoblastic leukaemia

Source: https://www.ema.europa.eu/en/medicines/human/orphan-designations/eu-3-05-338